EMA orphan designation EU/3/24/2982
Active substance: genetically modified human adenovirus encoding human PH20 hyaluronidase
Designated 2024-11-10. Orphan designation status: Positive.
First published: 2024-11-20.

Intended use: Treatment of retinoblastoma

Source: https://www.ema.europa.eu/en/medicines/human/orphan-designations/eu-3-24-2982